EMA orphan designation EU/3/15/1523
Active substance: Inecalcitol
Designated 2015-07-28. Orphan designation status: Positive.
First published: 2015-08-18. Last updated: 2015-08-18.

Intended use: Treatment of acute myeloid leukaemia

Source: https://www.ema.europa.eu/en/medicines/human/orphan-designations/eu-3-15-1523